EMA orphan designation EU/3/11/873
Active substance: human dermal fibroblasts cultured on a bioresorbable polyglactin mesh
Designated 2011-06-21. Orphan designation status: Withdrawn.
First published: 2011-07-12. Last updated: 2014-04-01.

Intended use: Treatment of epidermolysis bullosa

Source: https://www.ema.europa.eu/en/medicines/human/orphan-designations/eu-3-11-873